EMA orphan designation EU/3/03/176
Active substance: recombinant antibody derivative against human CD19 and CD3 (blinatumomab)
Designated 2003-12-01. Orphan designation status: Withdrawn.
First published: 2006-01-04. Last updated: 2020-12-11.

Intended use: Treatment of chronic lymphocytic leukaemia

Source: https://www.ema.europa.eu/en/medicines/human/orphan-designations/eu-3-03-176